EMA orphan designation EU/3/05/286
Active substance: N-(methyl-diazacyclohexyl-methylbenzamide)-azaphenyl-aminothiopyrrole
Designated 2005-06-20. Orphan designation status: Positive.
First published: 2005-07-26. Last updated: 2005-07-26.

Intended use: Treatment of multiple myeloma

Source: https://www.ema.europa.eu/en/medicines/human/orphan-designations/eu-3-05-286